EMA orphan designation EU/3/08/547
Active substance: anti-von Willebrand aptamer
Designated 2008-06-03. Orphan designation status: Withdrawn.
First published: 2008-07-29. Last updated: 2010-11-18.

Intended use: Treatment of thrombotic thrombocytopenic purpura

Source: https://www.ema.europa.eu/en/medicines/human/orphan-designations/eu-3-08-547